EMA orphan designation EU/3/17/1965
Active substance: metformin; l-citrulline
Designated 2018-01-17. Orphan designation status: Positive.
First published: 2018-03-12. Last updated: 2019-07-30.

Intended use: Treatment of Duchenne muscular dystrophy

Source: https://www.ema.europa.eu/en/medicines/human/orphan-designations/eu-3-17-1965